EMA orphan designation EU/3/18/2004
Active substance: tazemetostat
Designated 2018-03-21. Orphan designation status: Withdrawn.
First published: 2018-05-08. Last updated: 2026-05-18.

Intended use: Treatment of diffuse large B-cell lymphoma

Source: https://www.ema.europa.eu/en/medicines/human/orphan-designations/eu-3-18-2004